EMA orphan designation EU/3/18/2069
Active substance: tilorone
Designated 2018-08-24. Orphan designation status: Positive.
First published: 2018-12-19.

Intended use: Treatment of idiopathic pulmonary fibrosis

Source: https://www.ema.europa.eu/en/medicines/human/orphan-designations/eu-3-18-2069